EMA orphan designation EU/3/25/3069
Active substance: Humanised IgG4 bispecific monoclonal antibody against sclerostin and dickkopf-related protein 1
Designated 2025-06-20. Orphan designation status: Positive.
First published: 2025-07-25.

Intended use: Treatment of osteogenesis imperfecta

Source: https://www.ema.europa.eu/en/medicines/human/orphan-designations/eu-3-25-3069